EMA orphan designation EU/3/14/1243
Active substance: recombinant human acid ceramidase
Designated 2014-02-19. Orphan designation status: Positive.
First published: 2014-04-02. Last updated: 2021-06-25.

Intended use: Treatment of Farber disease

Source: https://www.ema.europa.eu/en/medicines/human/orphan-designations/eu-3-14-1243